EMA orphan designation EU/3/21/2540
Active substance: 3-(ethoxydifluoromethyl)-6-(5-fluoro-6-(2,2,2-trifluoroethoxy)pyridin-3-yl)-[1,2,4]triazolo[4,3-a]pyrazine
Designated 2021-12-10. Orphan designation status: Positive.
First published: 2022-04-19.

Intended use: Treatment of SCN8A developmental and epileptic encephalopathy

Source: https://www.ema.europa.eu/en/medicines/human/orphan-designations/eu-3-21-2540